EMA orphan designation EU/3/08/577
Active substance: carglumic acid
Designated 2008-11-07. Orphan designation status: Expired.
First published: 2009-04-02. Last updated: 2021-06-09.

Intended use: Treatment of propionic acidaemia

Source: https://www.ema.europa.eu/en/medicines/human/orphan-designations/eu-3-08-577